EMA orphan designation EU/3/15/1530
Active substance: beloranib
Orphan designation status: Withdrawn.
First published: 2015-08-18. Last updated: 2017-05-17.

Intended use: Treatment of craniopharyngioma

Source: https://www.ema.europa.eu/en/medicines/human/orphan-designations/eu-3-15-1530